EMA orphan designation EU/3/21/2546
Active substance: 6-(4-(tert-butyl)phenoxy)pyridin-3-amine
Designated 2021-12-10. Orphan designation status: Withdrawn.
First published: 2022-04-28. Last updated: 2024-11-19.

Intended use: Treatment of acute lymphoblastic leukaemia

Source: https://www.ema.europa.eu/en/medicines/human/orphan-designations/eu-3-21-2546